EMA orphan designation EU/3/22/2692
Active substance: Oxygen, sodium chloride solution 0.9%
Designated 2022-10-11. Orphan designation status: Positive.
First published: 2023-01-24. Last updated: 2024-04-08.

Intended use: Treatment of amyotrophic lateral sclerosis

Source: https://www.ema.europa.eu/en/medicines/human/orphan-designations/eu-3-22-2692